EMA orphan designation EU/3/20/2271: Zemcelpro
Active substance: haematopoietic stem cells and blood progenitors umbilical cord-derived expanded with (1R, 4R)-N1-(2-benzyl-7-(2-methyl-2H-tetrazol-5-yl)-9H-pyrimido[4,5-b]indol-4-yl)cyclohexane-1,4-diamine dihydrobromide dihydrate
Designated 2020-04-20. Orphan designation status: Positive. EMA product number: EMEA/H/C/005772.
First published: 2020-08-12. Last updated: 2025-06-20.

Intended use: Treatment in haematopoietic stem cell transplantation

Source: https://www.ema.europa.eu/en/medicines/human/orphan-designations/eu-3-20-2271